EMA orphan designation EU/3/18/2098
Active substance: Larotrectinib
Designated 2018-11-19. Orphan designation status: Withdrawn.
First published: 2019-02-22. Last updated: 2019-10-24.

Intended use: Treatment of papillary thyroid cancer

Source: https://www.ema.europa.eu/en/medicines/human/orphan-designations/eu-3-18-2098